EMA orphan designation EU/3/18/2013: Polivy
Active substance: polatuzumab vedotin
Designated 2018-04-16. Orphan designation status: Positive. EMA product number: EMEA/H/C/004870.
First published: 2018-05-17. Last updated: 2022-06-08.

Intended use: Treatment of diffuse large B-cell lymphoma

Source: https://www.ema.europa.eu/en/medicines/human/orphan-designations/eu-3-18-2013